EMA orphan designation EU/3/05/282
Active substance: Miltefosine
Designated 2005-05-27. Orphan designation status: Positive.
First published: 2009-09-23. Last updated: 2016-02-09.

Intended use: Treatment of acanthamoeba keratitis

Source: https://www.ema.europa.eu/en/medicines/human/orphan-designations/eu-3-05-282